EMA orphan designation EU/3/05/287
Active substance: bovine bile extract
Designated 2005-06-20. Orphan designation status: Positive.
First published: 2007-03-07. Last updated: 2022-03-18.

Intended use: Treatment of pancreatic cancer

Source: https://www.ema.europa.eu/en/medicines/human/orphan-designations/eu-3-05-287